EMA orphan designation EU/3/11/916
Active substance: 2-Hydroxyoleic acid
Designated 2011-10-27. Orphan designation status: Positive.
First published: 2011-11-23. Last updated: 2021-02-03.

Intended use: Treatment of glioma

Source: https://www.ema.europa.eu/en/medicines/human/orphan-designations/eu-3-11-916